EMA orphan designation EU/3/14/1413
Active substance: allogeneic, umbilical cord blood-derived, ex vivo-expanded, haematopoietic CD133+ cells; allogeneic, umbilical cord blood-derived, non-expanded, haematopoietic CD133- cells
Designated 2015-01-15. Orphan designation status: Withdrawn.
First published: 2015-03-05. Last updated: 2018-01-23.

Intended use: Treatment of acute myeloid leukaemia

Source: https://www.ema.europa.eu/en/medicines/human/orphan-designations/eu-3-14-1413